EMA orphan designation EU/3/16/1743
Active substance: autologous mononuclear cells derived from human cord blood
Designated 2016-10-14. Orphan designation status: Positive.
First published: 2016-11-15. Last updated: 2016-11-15.

Intended use: Treatment of neonatal encephalopathy

Source: https://www.ema.europa.eu/en/medicines/human/orphan-designations/eu-3-16-1743